EMA orphan designation EU/3/17/1860
Active substance: Poly-cyclodextrin-bis-cysteine-PEG3400-camptothecin-conjugate
Designated 2017-03-20. Orphan designation status: Positive.
First published: 2017-05-05. Last updated: 2017-05-05.

Intended use: Treatment of ovarian cancer

Source: https://www.ema.europa.eu/en/medicines/human/orphan-designations/eu-3-17-1860